EMA orphan designation EU/3/10/804
Active substance: Methylthioninium
Designated 2010-11-26. Orphan designation status: Positive.
First published: 2010-12-15. Last updated: 2019-03-28.

Intended use: Treatment of progressive supranuclear palsy

Source: https://www.ema.europa.eu/en/medicines/human/orphan-designations/eu-3-10-804